EMA orphan designation EU/3/11/855
Active substance: recombinant fusion protein linking human coagulation factor VIIa with human albumin
Designated 2011-04-15. Orphan designation status: Positive.
First published: 2011-04-26. Last updated: 2018-03-28.

Intended use: Treatment of haemophilia A

Source: https://www.ema.europa.eu/en/medicines/human/orphan-designations/eu-3-11-855